EMA orphan designation EU/3/24/2957
Active substance: adeno-associated virus vector serotype rh.10 containing the human FXN gene
Designated 2024-07-25. Orphan designation status: Positive.
First published: 2024-09-13.

Intended use: Treatment of Friedreich's ataxia

Source: https://www.ema.europa.eu/en/medicines/human/orphan-designations/eu-3-24-2957